EMA orphan designation EU/3/20/2289
Active substance: nomacopan
Designated 2020-06-26. Orphan designation status: Positive.
First published: 2020-09-22. Last updated: 2022-01-26.

Intended use: Treatment of bullous pemphigoid

Source: https://www.ema.europa.eu/en/medicines/human/orphan-designations/eu-3-20-2289